EMA orphan designation EU/3/15/1501
Active substance: adeno-associated viral vector containing the human factor IX gene
Designated 2015-06-19. Orphan designation status: Withdrawn.
First published: 2015-07-27. Last updated: 2017-10-19.

Intended use: Treatment of haemophilia B

Source: https://www.ema.europa.eu/en/medicines/human/orphan-designations/eu-3-15-1501